EMA orphan designation EU/3/01/062
Active substance: idebenone
Designated 2001-11-20. Orphan designation status: Positive.
First published: 2005-10-11.

Intended use: Treatment of Friedreich's ataxia

Source: https://www.ema.europa.eu/en/medicines/human/orphan-designations/eu-3-01-062